EMA orphan designation EU/3/18/1972
Active substance: 1-[[[4-(4-Fluoro-2-methyl-1H-indol-5-yloxy)-6-methoxyquinolin-7-yl]oxy]methyl]cyclopropanamine-dihydrochloride
Designated 2018-02-22. Orphan designation status: Positive.
First published: 2018-04-11. Last updated: 2018-04-11.

Intended use: Treatment of soft tissue sarcoma

Source: https://www.ema.europa.eu/en/medicines/human/orphan-designations/eu-3-18-1972